EMA orphan designation EU/3/13/1209
Active substance: nitric oxide
Designated 2014-01-16. Orphan designation status: Positive.
First published: 2014-01-23. Last updated: 2014-01-23.

Intended use: Treatment of cystic fibrosis

Source: https://www.ema.europa.eu/en/medicines/human/orphan-designations/eu-3-13-1209